EMA orphan designation EU/3/02/101
Active substance: pseudomonas exotoxin (domains II/III)-interleukin 13 chimeric protein
Designated 2002-04-30. Orphan designation status: Withdrawn.
First published: 2007-12-04. Last updated: 2016-10-20.

Intended use: Treatment of glioma

Source: https://www.ema.europa.eu/en/medicines/human/orphan-designations/eu-3-02-101